EMA orphan designation EU/3/23/2862
Active substance: allogeneic umbilical cord-derived mesenchymal stromal cells, pooled
Designated 2023-12-13. Orphan designation status: Positive.
First published: 2024-02-01. Last updated: 2024-10-11.

Intended use: Treatment of perinatal asphyxia

Source: https://www.ema.europa.eu/en/medicines/human/orphan-designations/eu-3-23-2862